EMA orphan designation EU/3/20/2280
Active substance: Lutetium (177Lu) lilotomab satetraxetan
Designated 2020-06-04. Orphan designation status: Positive.
First published: 2020-08-19.

Intended use: Treatment of marginal zone lymphoma

Source: https://www.ema.europa.eu/en/medicines/human/orphan-designations/eu-3-20-2280